EMA orphan designation EU/3/16/1781
Active substance: live-attenuated non-replicative Pseudomonas aeruginosa strain expressing large T antigen of Merkel cell polyomavirus
Designated 2016-11-18. Orphan designation status: Positive.
First published: 2016-12-14. Last updated: 2016-12-14.

Intended use: Treatment of Merkel cell carcinoma

Source: https://www.ema.europa.eu/en/medicines/human/orphan-designations/eu-3-16-1781